EMA orphan designation EU/3/26/3208
Active substance: adeno-associated viral vector serotype 44.9 containing the human HRS1 gene
Designated 2026-02-23. Orphan designation status: Positive.
First published: 2026-04-14.

Intended use: Treatment of inherited retinal dystrophy due to dysfunction in the human retinoschisin (RS1) gene

Source: https://www.ema.europa.eu/en/medicines/human/orphan-designations/eu-3-26-3208